EMA orphan designation EU/3/14/1314
Active substance: recombinant human apolipoprotein A-I in a complex with phospholipids
Designated 2014-08-22. Orphan designation status: Positive.
First published: 2014-09-30. Last updated: 2014-09-30.

Intended use: Treatment of ATP-binding cassette transporter A1 deficiency

Source: https://www.ema.europa.eu/en/medicines/human/orphan-designations/eu-3-14-1314